EMA orphan designation EU/3/23/2886
Active substance: human coagulation factor X
Designated 2024-01-12. Orphan designation status: Positive.
First published: 2024-04-11.

Intended use: Treatment of acquired factor X deficiency

Source: https://www.ema.europa.eu/en/medicines/human/orphan-designations/eu-3-23-2886